EMA orphan designation EU/3/20/2292
Active substance: anti-CD123 IgG1 humanised monoclonal antibody conjugated to N1-(2-(2,5-dioxo-2,5-dihydro-1H-pyrrol-1-yl)ethyl)-N6-((S)-1-(((S)-1-((3-((((S)-8-methoxy-6-oxo-11,12,12a,13-tetrahydro-6H-benzo[5,6][1,4]diazepino[1,2-a]indol-9-yl)oxy)methyl)-5-((((S)-8-methoxy-6-oxo-12a,13-dihydro-6Hbenzo[5,6][1,4]diazepino[1,2-a]indol-9-yl)oxy)methyl)phenyl)amino)-1-oxopropan-2-yl)amino)-1-oxopropan-2-yl)adipamide; pivekimab sunirine
Designated 2020-06-26. Orphan designation status: Positive.
First published: 2020-09-23. Last updated: 2024-10-22.

Intended use: Treatment of blastic plasmacytoid dendritic cell neoplasm

Source: https://www.ema.europa.eu/en/medicines/human/orphan-designations/eu-3-20-2292